EMA orphan designation EU/3/09/650: Blincyto
Active substance: blinatumomab
Designated 2009-07-24. Orphan designation status: Positive. EMA product number: EMEA/H/C/003731.
First published: 2009-09-11. Last updated: 2015-12-08.

Intended use: Treatment of acute lymphoblastic leukaemia

Source: https://www.ema.europa.eu/en/medicines/human/orphan-designations/eu-3-09-650